EMA orphan designation EU/3/22/2731: Hetronifly
Active substance: serplulimab
Orphan designation status: Withdrawn. EMA product number: EMEA/H/C/006170.
First published: 2023-03-13. Last updated: 2026-05-18.

Intended use: Treatment of small cell lung cancer

Source: https://www.ema.europa.eu/en/medicines/human/orphan-designations/eu-3-22-2731